EMA orphan designation EU/3/22/2746
Active substance: adeno-associated viral vector serotype 2 containing the human SLC6A3 gene
Designated 2023-01-13. Orphan designation status: Positive.
First published: 2023-03-23.

Intended use: Treatment of dopamine transporter deficiency syndrome

Source: https://www.ema.europa.eu/en/medicines/human/orphan-designations/eu-3-22-2746